EMA orphan designation EU/3/16/1778: Kinpeygo
Active substance: Budesonide
Designated 2016-11-18. Orphan designation status: Positive. EMA product number: EMEA/H/C/005653.
First published: 2016-12-13. Last updated: 2022-09-14.

Intended use: Treatment of primary IgA nephropathy

Source: https://www.ema.europa.eu/en/medicines/human/orphan-designations/eu-3-16-1778